EMA orphan designation EU/3/16/1817
Active substance: human donor haematopoietic stem and progenitor cells that have been treated ex vivo with the protein transduction domain of the HIV-1 transactivation protein fused to MYC transcription factor
Designated 2017-01-12. Orphan designation status: Positive.
First published: 2017-07-11. Last updated: 2018-06-12.

Intended use: Treatment in haematopoietic stem cell transplantation

Source: https://www.ema.europa.eu/en/medicines/human/orphan-designations/eu-3-16-1817